EMA orphan designation EU/3/18/2106
Active substance: 6-fluoro-9-methyl-9H-pyrido[3,4-b]-indole
Designated 2018-12-14. Orphan designation status: Positive.
First published: 2019-03-12.

Intended use: Treatment of sudden sensorineural hearing loss

Source: https://www.ema.europa.eu/en/medicines/human/orphan-designations/eu-3-18-2106